EMA orphan designation EU/3/24/2961
Active substance: obrixtamig
Designated 2024-08-21. Orphan designation status: Positive.
First published: 2024-09-19.

Intended use: Treatment of pulmonary neuroendocrine carcinoma

Source: https://www.ema.europa.eu/en/medicines/human/orphan-designations/eu-3-24-2961